EMA orphan designation EU/3/23/2802
Active substance: lusvertikimab
Designated 2023-07-25. Orphan designation status: Positive.
First published: 2024-01-26.

Intended use: Treatment of acute lymphoblastic leukaemia

Source: https://www.ema.europa.eu/en/medicines/human/orphan-designations/eu-3-23-2802